EMA orphan designation EU/3/17/1851
Active substance: Adeno-associated viral vector serotype rh.10 expressing beta-galactosidase
Designated 2017-03-20. Orphan designation status: Positive.
First published: 2017-05-05. Last updated: 2017-05-05.

Intended use: Treatment of GM1 gangliosidosis

Source: https://www.ema.europa.eu/en/medicines/human/orphan-designations/eu-3-17-1851